EMA orphan designation EU/3/08/596: Adcetris
Active substance: monoclonal antibody against human CD30 covalently linked to the cytotoxin monomethylauristatin E (brentuximab vedotin)
Designated 2009-01-15. Orphan designation status: Withdrawn. EMA product number: EMEA/H/C/002455.
First published: 2009-05-15. Last updated: 2024-06-26.

Intended use: Treatment of Hodgkin's lymphoma

Source: https://www.ema.europa.eu/en/medicines/human/orphan-designations/eu-3-08-596